EMA orphan designation EU/3/13/1171
Active substance: autologous regulatory T cells with an immunophenotype of CD4+CD25hiFoxP3+
Designated 2013-10-08. Orphan designation status: Positive.
First published: 2013-12-03. Last updated: 2013-12-03.

Intended use: Prevention of rejection following solid organ transplantation

Source: https://www.ema.europa.eu/en/medicines/human/orphan-designations/eu-3-13-1171